EMA orphan designation EU/3/04/226
Active substance: recombinant human interleukin-21
Designated 2004-09-02. Orphan designation status: Withdrawn.
First published: 2004-10-01. Last updated: 2014-10-16.

Intended use: Treatment of renal-cell carcinoma

Source: https://www.ema.europa.eu/en/medicines/human/orphan-designations/eu-3-04-226